EMA orphan designation EU/3/19/2236
Active substance: dimethyl fumarate
Designated 2020-01-09. Orphan designation status: Positive.
First published: 2020-05-14. Last updated: 2022-11-11.

Intended use: Treatment of adrenoleukodystrophy

Source: https://www.ema.europa.eu/en/medicines/human/orphan-designations/eu-3-19-2236